EMA orphan designation EU/3/05/277
Active substance: 3-[5-(2-Fluoro-phenyl)-[1,2,4]oxadiazole-3-yl]-benzoic acid
Designated 2005-05-27. Orphan designation status: Withdrawn.
First published: 2007-07-04. Last updated: 2017-05-17.

Intended use: Treatment of cystic fibrosis

Source: https://www.ema.europa.eu/en/medicines/human/orphan-designations/eu-3-05-277